EMA orphan designation EU/3/03/141
Active substance: 2-Chloro-9-[2-deoxy-2-fluoro-ß-D-arabinofuranosyl]adenine
Designated 2003-05-08. Orphan designation status: Withdrawn.
First published: 2008-08-18. Last updated: 2014-10-16.

Intended use: Treatment of acute myeloid leukaemia

Source: https://www.ema.europa.eu/en/medicines/human/orphan-designations/eu-3-03-141